EMA orphan designation EU/3/12/1074
Active substance: alisertib
Designated 2012-12-06. Orphan designation status: Withdrawn.
First published: 2013-01-24. Last updated: 2017-02-07.

Intended use: Treatment of peripheral T-cell lymphoma (nodal, other extranodal and leukaemic/disseminated)

Source: https://www.ema.europa.eu/en/medicines/human/orphan-designations/eu-3-12-1074